EMA orphan designation EU/3/24/2922
Active substance: adeno-associated virus serotype 9 containing CRISPR/Cas13Y and guide RNA against the human MECP2 gene
Designated 2024-05-24. Orphan designation status: Positive.
First published: 2024-08-22.

Intended use: Treatment of MECP2 duplication syndrome

Source: https://www.ema.europa.eu/en/medicines/human/orphan-designations/eu-3-24-2922